EMA orphan designation EU/3/12/1078
Active substance: exon 55 specific phosphorothioate oligonucleotide
Designated 2012-12-06. Orphan designation status: Positive.
First published: 2013-01-25. Last updated: 2016-12-05.

Intended use: Treatment of Duchenne muscular dystrophy

Source: https://www.ema.europa.eu/en/medicines/human/orphan-designations/eu-3-12-1078